EMA orphan designation EU/3/22/2602
Active substance: Devimistat
Designated 2022-04-13. Orphan designation status: Positive.
First published: 2022-07-19.

Intended use: Treatment of biliary tract cancer

Source: https://www.ema.europa.eu/en/medicines/human/orphan-designations/eu-3-22-2602